EMA orphan designation EU/3/22/2684
Active substance: batoclimab
Designated 2022-08-10. Orphan designation status: Positive.
First published: 2022-12-15. Last updated: 2024-04-11.

Intended use: Treatment of myasthenia gravis

Source: https://www.ema.europa.eu/en/medicines/human/orphan-designations/eu-3-22-2684